EMA orphan designation EU/3/06/351
Active substance: miglustat
Designated 2006-02-16. Orphan designation status: Expired.
First published: 2006-08-24. Last updated: 2019-02-20.

Intended use: Treatment of Niemann-Pick's disease, type C

Source: https://www.ema.europa.eu/en/medicines/human/orphan-designations/eu-3-06-351